EMA orphan designation EU/3/16/1637: Oxlumo
Active substance: synthetic double-stranded siRNA oligonucleotide directed against hydroxyacid oxidase 1 mRNA and covalently linked to a ligand containing three N-acetylgalactosamine residues (lumasiran)
Designated 2016-03-21. Orphan designation status: Positive. EMA product number: EMEA/H/C/005040.
First published: 2016-05-02. Last updated: 2020-11-25.

Intended use: Treatment of primary hyperoxaluria

Source: https://www.ema.europa.eu/en/medicines/human/orphan-designations/eu-3-16-1637